EMA orphan designation EU/3/02/096
Active substance: nitisinone
Designated 2002-03-13. Orphan designation status: Withdrawn.
First published: 2003-01-06. Last updated: 2020-04-01.

Intended use: Treatment of alkaptonuria

Source: https://www.ema.europa.eu/en/medicines/human/orphan-designations/eu-3-02-096